EMA orphan designation EU/3/13/1129
Active substance: allogeneic bone-marrow-derived mesenchymal cells expanded ex vivo in synthetic media
Designated 2013-06-07. Orphan designation status: Positive.
First published: 2013-06-25. Last updated: 2013-07-09.

Intended use: Treatment of graft-versus-host disease

Source: https://www.ema.europa.eu/en/medicines/human/orphan-designations/eu-3-13-1129